EMA orphan designation EU/3/11/921
Active substance: Glycosylation independent lysosomal targeting (GILT)-tagged recombinant human acid alpha glucosidase
Designated 2011-10-27. Orphan designation status: Positive.
First published: 2011-11-23. Last updated: 2021-11-30.

Intended use: Treatment of glycogen storage disease type II (Pompe's disease)

Source: https://www.ema.europa.eu/en/medicines/human/orphan-designations/eu-3-11-921